EMA orphan designation EU/3/03/173
Active substance: vasoactive Intestinal Peptide
Designated 2003-12-22. Orphan designation status: Positive.
First published: 2009-09-23. Last updated: 2010-06-23.

Intended use: Treatment of pulmonary arterial hypertension and chronic thromboembolic pulmonary hypertension

Source: https://www.ema.europa.eu/en/medicines/human/orphan-designations/eu-3-03-173